EMA orphan designation EU/3/24/2951
Active substance: befiradol fumarate
Designated 2024-07-25. Orphan designation status: Positive.
First published: 2024-09-13.

Intended use: Treatment of spinocerebellar ataxia

Source: https://www.ema.europa.eu/en/medicines/human/orphan-designations/eu-3-24-2951